EMA orphan designation EU/3/04/189: Sovrima
Active substance: idebenone
Designated 2004-03-08. Orphan designation status: Positive. EMA product number: EMEA/H/C/000908.
First published: 2009-02-10. Last updated: 2024-10-22.

Intended use: Treatment of Friedreich's ataxia

Source: https://www.ema.europa.eu/en/medicines/human/orphan-designations/eu-3-04-189